EMA orphan designation EU/3/12/1004
Active substance: ramucirumab
Designated 2012-07-04. Orphan designation status: Withdrawn.
First published: 2012-07-24. Last updated: 2016-01-19.

Intended use: Treatment of gastric cancer

Source: https://www.ema.europa.eu/en/medicines/human/orphan-designations/eu-3-12-1004